EMA orphan designation EU/3/12/975
Active substance: carbetocin
Designated 2012-03-21. Orphan designation status: Positive.
First published: 2012-04-19. Last updated: 2021-10-13.

Intended use: Treatment of Prader-Willi syndrome

Source: https://www.ema.europa.eu/en/medicines/human/orphan-designations/eu-3-12-975